EMA orphan designation EU/3/17/1930: Lyvdelzi (previously Seladelpar Gilead)
Active substance: seladelpar
Orphan designation status: Positive. EMA product number: EMEA/H/C/004692.
First published: 2018-01-10. Last updated: 2025-03-12.

Intended use: Treatment of primary biliary cholangitis

Source: https://www.ema.europa.eu/en/medicines/human/orphan-designations/eu-3-17-1930